EMA orphan designation EU/3/10/841
Active substance: tasimelteon
Designated 2011-02-23. Orphan designation status: Expired.
First published: 2011-03-07. Last updated: 2025-07-09.

Intended use: Treatment of non-24-hour sleep-wake disorders in blind people with no light perception

Source: https://www.ema.europa.eu/en/medicines/human/orphan-designations/eu-3-10-841